EMA orphan designation EU/3/18/2015
Active substance: adeno-associated viral vector serotype 8 containing a functional copy of the codon-optimised F8 cDNA encoding the B-domain deleted human coagulation factor VIII
Designated 2018-05-25. Orphan designation status: Withdrawn.
First published: 2018-07-18. Last updated: 2024-04-09.

Intended use: Treatment of haemophilia A

Source: https://www.ema.europa.eu/en/medicines/human/orphan-designations/eu-3-18-2015